EMA orphan designation EU/3/22/2633
Active substance: sirolimus
Designated 2022-06-21. Orphan designation status: Positive.
First published: 2022-09-22.

Intended use: Treatment of lymphatic malformations

Source: https://www.ema.europa.eu/en/medicines/human/orphan-designations/eu-3-22-2633